EMA orphan designation EU/3/26/3229
Active substance: antisense oligonucleotide against SYNGAP1 regulatory RNA
Designated 2026-04-20. Orphan designation status: Positive.
First published: 2026-04-22.

Intended use: Treatment of SYNGAP1-related disorder

Source: https://www.ema.europa.eu/en/medicines/human/orphan-designations/eu-3-26-3229